EMA orphan designation EU/3/21/2468
Active substance: melatonin
Designated 2021-07-19. Orphan designation status: Positive.
First published: 2021-11-12.

Intended use: Treatment of non-traumatic spontaneous intracerebral haemorrhage

Source: https://www.ema.europa.eu/en/medicines/human/orphan-designations/eu-3-21-2468